EMA orphan designation EU/3/16/1695
Active substance: autologous Epstein-Barr virus specific T cells derived from peripheral blood mononuclear cells, expanded ex vivo
Designated 2016-07-14. Orphan designation status: Withdrawn.
First published: 2016-09-06. Last updated: 2020-05-25.

Intended use: Treatment of extranodal NK/T-cell lymphoma, nasal type

Source: https://www.ema.europa.eu/en/medicines/human/orphan-designations/eu-3-16-1695